EMA orphan designation EU/3/25/3038
Active substance: adeno-associated virus vector serotype 8 containing the human F9 gene
Designated 2025-03-25. Orphan designation status: Positive.
First published: 2025-04-07.

Intended use: Treatment of haemophilia B

Source: https://www.ema.europa.eu/en/medicines/human/orphan-designations/eu-3-25-3038